EMA orphan designation EU/3/19/2160
Active substance: allogeneic skin-derived ABCB5-positive mesenchymal stem cells
Designated 2019-05-29. Orphan designation status: Positive.
First published: 2019-08-09.

Intended use: Treatment of epidermolysis bullosa

Source: https://www.ema.europa.eu/en/medicines/human/orphan-designations/eu-3-19-2160